EMA orphan designation EU/3/04/228
Active substance: Homoharringtonine
Designated 2004-10-20. Orphan designation status: Withdrawn.
First published: 2009-09-21. Last updated: 2016-08-10.

Intended use: Treatment of acute myeloid leukaemia

Source: https://www.ema.europa.eu/en/medicines/human/orphan-designations/eu-3-04-228